EMA orphan designation EU/3/05/348
Active substance: purified inactivated Japanese encephalitis SA14-4-2 virus vaccine
Designated 2006-01-24. Orphan designation status: Withdrawn.
First published: 2009-09-23. Last updated: 2009-09-23.

Intended use: Prevention of Japanese encephalitis

Source: https://www.ema.europa.eu/en/medicines/human/orphan-designations/eu-3-05-348